EMA orphan designation EU/3/16/1790
Active substance: Tyr-Gly-Arg-Lys-Lys-Arg-Arg-Gln-Arg-Arg-Gly-Gly-Asp-Leu-Leu-Pro-Arg-Gly-Ser
Designated 2016-11-18. Orphan designation status: Positive.
First published: 2016-12-14. Last updated: 2022-03-18.

Intended use: Treatment of Huntington’s disease

Source: https://www.ema.europa.eu/en/medicines/human/orphan-designations/eu-3-16-1790